EMA orphan designation EU/3/13/1138
Active substance: copper meso-5,15-bis[3-[(1,2-dicarba-closo-dodecaboranyl)methoxy]phenyl]-meso-10,20-dinitroporphyrin
Designated 2013-06-27. Orphan designation status: Withdrawn.
First published: 2013-07-11. Last updated: 2020-12-10.

Intended use: Treatment of squamous-cell carcinoma of the head and neck in patients undergoing radiotherapy

Source: https://www.ema.europa.eu/en/medicines/human/orphan-designations/eu-3-13-1138